EMA orphan designation EU/3/21/2473
Active substance: adeno-associated viral vector serotype 9 containing the human SLC13A5 gene
Designated 2021-07-19. Orphan designation status: Positive.
First published: 2021-11-16.

Intended use: Treatment of SLC13A5-epileptic encephalopathy deficiencies

Source: https://www.ema.europa.eu/en/medicines/human/orphan-designations/eu-3-21-2473